EMA orphan designation EU/3/15/1459
Active substance: Enoxacin
Designated 2015-03-19. Orphan designation status: Positive.
First published: 2015-05-11. Last updated: 2021-09-02.

Intended use: Treatment of amyotrophic lateral sclerosis

Source: https://www.ema.europa.eu/en/medicines/human/orphan-designations/eu-3-15-1459